EMA orphan designation EU/3/19/2137
Active substance: allogeneic cultured postnatal thymus-derived tissue
Designated 2019-02-26. Orphan designation status: Withdrawn.
First published: 2019-04-09.

Intended use: Treatment of severe combined immunodeficiency due to FOXN1 deficiency

Source: https://www.ema.europa.eu/en/medicines/human/orphan-designations/eu-3-19-2137